EMA orphan designation EU/3/12/1095
Active substance: adeno-associated viral vector serotype 9 containing the human N-acetylglucosaminidase-alpha gene
Designated 2013-01-24. Orphan designation status: Withdrawn.
First published: 2013-03-01. Last updated: 2025-02-20.

Intended use: Treatment of mucopolysaccharidosis type IIIB (Sanfilippo B syndrome)

Source: https://www.ema.europa.eu/en/medicines/human/orphan-designations/eu-3-12-1095